EMA orphan designation EU/3/10/795
Active substance: pralatrexate
Designated 2010-10-01. Orphan designation status: Positive.
First published: 2010-10-14. Last updated: 2010-10-14.

Intended use: Treatment of Hodgkin's lymphoma

Source: https://www.ema.europa.eu/en/medicines/human/orphan-designations/eu-3-10-795